EMA orphan designation EU/3/16/1651
Active substance: recombinant adeno-associated viral vector serotype 9 carrying the gene for the human E6-AP ubiquitin protein ligase
Designated 2016-04-28. Orphan designation status: Positive.
First published: 2016-05-30. Last updated: 2018-12-18.

Intended use: Treatment of Angelman syndrome

Source: https://www.ema.europa.eu/en/medicines/human/orphan-designations/eu-3-16-1651